EMA orphan designation EU/3/12/1001
Active substance: 1-(4-{4-Amino-7-[1-(2-hydroxyethyl)-1H-pyrazol-4-yl]thieno[3,2-c]pyridin-3-yl}phenyl)-3-(3-fluorophenyl)urea
Designated 2012-06-06. Orphan designation status: Withdrawn.
First published: 2012-07-04. Last updated: 2015-06-08.

Intended use: Treatment of ovarian cancer

Source: https://www.ema.europa.eu/en/medicines/human/orphan-designations/eu-3-12-1001